EMA orphan designation EU/3/05/306
Active substance: imatinib mesilate
Designated 2005-08-26. Orphan designation status: Withdrawn.
First published: 2007-12-04. Last updated: 2015-02-10.

Intended use: Treatment of mastocytosis

Source: https://www.ema.europa.eu/en/medicines/human/orphan-designations/eu-3-05-306